EMA orphan designation EU/3/20/2293
Designated 2020-06-26. Orphan designation status: Withdrawn.
First published: 2020-09-23. Last updated: 2024-11-19.

Intended use: Treatment of Becker muscular dystrophy

Source: https://www.ema.europa.eu/en/medicines/human/orphan-designations/eu-3-20-2293